EMA orphan designation EU/3/23/2769
Active substance: Indole-3-carboxaldehyde
Designated 2023-03-20. Orphan designation status: Positive.
First published: 2024-02-01.

Intended use: Treatment of primary CTLA-4 checkpoint related immunodeficiencies

Source: https://www.ema.europa.eu/en/medicines/human/orphan-designations/eu-3-23-2769